EMA orphan designation EU/3/15/1603
Active substance: Live attenuated Listeria monocytogenes delta actA/delta inlB strain expressing human mesothelin
Designated 2016-01-11. Orphan designation status: Withdrawn.
First published: 2016-02-05. Last updated: 2020-01-13.

Intended use: Treatment of pancreatic cancer

Source: https://www.ema.europa.eu/en/medicines/human/orphan-designations/eu-3-15-1603